EMA orphan designation EU/3/12/1083
Active substance: Humanised single-chain monoclonal antibody against CD37 (otlertuzumab)
Designated 2012-12-06. Orphan designation status: Withdrawn.
First published: 2013-01-25. Last updated: 2021-03-19.

Intended use: Treatment chronic lymphocytic leukaemia

Source: https://www.ema.europa.eu/en/medicines/human/orphan-designations/eu-3-12-1083